EMA orphan designation EU/3/18/2034: Upkanz
Active substance: Deferiprone
Designated 2018-06-27. Orphan designation status: Positive. EMA product number: EMEA/H/C/005004.
First published: 2018-08-21. Last updated: 2020-02-04.

Intended use: Treatment of neurodegeneration with brain iron accumulation

Source: https://www.ema.europa.eu/en/medicines/human/orphan-designations/eu-3-18-2034